EMA orphan designation EU/3/14/1287
Active substance: beloranib
Designated 2014-07-04. Orphan designation status: Withdrawn.
First published: 2014-07-15. Last updated: 2017-05-17.

Intended use: Treatment of Prader-Willi syndrome

Source: https://www.ema.europa.eu/en/medicines/human/orphan-designations/eu-3-14-1287